EMA orphan designation EU/3/06/402
Active substance: opebacan
Designated 2006-08-28. Orphan designation status: Withdrawn.
First published: 2009-04-24. Last updated: 2009-04-24.

Intended use: Treatment of meningococcal disease

Source: https://www.ema.europa.eu/en/medicines/human/orphan-designations/eu-3-06-402